EMA orphan designation EU/3/16/1782
Active substance: L-selenomethionine
Designated 2016-11-18. Orphan designation status: Positive.
First published: 2016-12-14. Last updated: 2016-12-14.

Intended use: treatment of facioscapulohumeral muscular dystrophy

Source: https://www.ema.europa.eu/en/medicines/human/orphan-designations/eu-3-16-1782